EMA orphan designation EU/3/09/644
Active substance: Murine monoclonal antibody to GD2
Designated 2009-06-12. Orphan designation status: Withdrawn.
First published: 2009-07-01. Last updated: 2011-03-28.

Intended use: Treatment of neuroblastoma

Source: https://www.ema.europa.eu/en/medicines/human/orphan-designations/eu-3-09-644